EMA orphan designation EU/3/25/3191
Active substance: Adeno-associated virus serotype ATC-0187 vector containing the human BAG3 gene
Designated 2026-01-09. Orphan designation status: Positive.
First published: 2026-04-10.

Intended use: Treatment of BAG3 dilated cardiomyopathy

Source: https://www.ema.europa.eu/en/medicines/human/orphan-designations/eu-3-25-3191